EMA orphan designation EU/3/21/2507
Active substance: autologous haematopoietic stem and progenitor cell population containing CD34+ cells transduced with a lentiviral vector encoding the TCIRG1 cDNA ex vivo expanded
Designated 2021-10-15. Orphan designation status: Positive.
First published: 2022-01-21. Last updated: 2022-11-07.

Intended use: Treatment of osteopetrosis

Source: https://www.ema.europa.eu/en/medicines/human/orphan-designations/eu-3-21-2507